EMA orphan designation EU/3/16/1714
Active substance: 6'-(R)-methyl-5-O-(5-amino-5,6-dideoxy-α-L-talofuranosyl)-paromamine sulfate
Designated 2016-09-22. Orphan designation status: Positive.
First published: 2016-10-11. Last updated: 2024-04-11.

Intended use: Treatment of mucopolysaccharidosis type I

Source: https://www.ema.europa.eu/en/medicines/human/orphan-designations/eu-3-16-1714